EMA orphan designation EU/3/02/093: orBec
Active substance: beclomethasone 17, 21-dipropionate
Designated 2002-03-13. Orphan designation status: Positive. EMA product number: EMEA/H/C/000803.
First published: 2008-08-18. Last updated: 2020-06-08.

Intended use: Treatment of intestinal graft-versus-host disease

Source: https://www.ema.europa.eu/en/medicines/human/orphan-designations/eu-3-02-093